EMA orphan designation EU/3/12/1054
Active substance: obinutuzumab
Designated 2012-10-10. Orphan designation status: Positive.
First published: 2012-11-16. Last updated: 2018-02-21.

Intended use: Treatment of chronic lymphocytic leukaemia

Source: https://www.ema.europa.eu/en/medicines/human/orphan-designations/eu-3-12-1054